EMA orphan designation EU/3/11/849: Prevymis
Active substance: (S)-{8-Fluoro-2-2[4-(3-methoxyphenyl)-1-piperazinyl]-3-[2-methoxy-5-(trifluoromethyl)-phenyl]-3, 4-dihydro-4-quinazolinyl} acetic acid (letermovir)
Designated 2011-04-15. Orphan designation status: Positive. EMA product number: EMEA/H/C/004536.
First published: 2011-04-26. Last updated: 2018-06-28.

Intended use: Prevention of cytomegalovirus disease in patients with impaired cell-mediated immunity

Source: https://www.ema.europa.eu/en/medicines/human/orphan-designations/eu-3-11-849